EMA orphan designation EU/3/25/3186
Active substance: N-[5-(2H-1,3-Benzodioxol-5-yl)-6-{2-[(5-bromopyrimidin-2-yl)oxy]ethoxy}pyrimidin-4-yl]-N'-(2-methoxyethyl)sulfuric diamide
Designated 2026-01-09. Orphan designation status: Positive.
First published: 2026-04-10.

Intended use: Treatment of primary IgA nephropathy

Source: https://www.ema.europa.eu/en/medicines/human/orphan-designations/eu-3-25-3186